EMA orphan designation EU/3/22/2658
Active substance: heterologous swine glyco-humanised polyclonal antibody against T lymphocytes
Designated 2022-07-18. Orphan designation status: Positive.
First published: 2023-01-23.

Intended use: Treatment in solid organ transplantation

Source: https://www.ema.europa.eu/en/medicines/human/orphan-designations/eu-3-22-2658